EMA orphan designation EU/3/16/1634
Active substance: Glucopyranosyl lipid A stable emulsion and recombinant New York oesophageal squamous cell carcinoma-1 protein
Designated 2016-03-21. Orphan designation status: Withdrawn.
First published: 2016-05-02. Last updated: 2019-10-15.

Intended use: Treatment of soft tissue sarcoma

Source: https://www.ema.europa.eu/en/medicines/human/orphan-designations/eu-3-16-1634